EMA orphan designation EU/3/18/2127
Active substance: melatonin
Designated 2019-01-11. Orphan designation status: Positive.
First published: 2019-03-27. Last updated: 2019-06-28.

Intended use: Treatment of perinatal asphyxia

Source: https://www.ema.europa.eu/en/medicines/human/orphan-designations/eu-3-18-2127